EMA orphan designation EU/3/16/1633
Active substance: Fosbretabulin tromethamine
Designated 2016-03-21. Orphan designation status: Withdrawn.
First published: 2016-05-04. Last updated: 2021-09-02.

Intended use: Treatment of gastro-entero-pancreatic neuroendocrine tumours

Source: https://www.ema.europa.eu/en/medicines/human/orphan-designations/eu-3-16-1633